EMA orphan designation EU/3/01/047
Active substance: thalidomide
Designated 2001-07-09. Orphan designation status: Withdrawn.
First published: 2009-10-15. Last updated: 2009-10-15.

Intended use: Treatment of multiple myeloma

Source: https://www.ema.europa.eu/en/medicines/human/orphan-designations/eu-3-01-047